EMA orphan designation EU/3/11/908
Active substance: Kifunensine
Designated 2011-09-27. Orphan designation status: Withdrawn.
First published: 2011-10-17. Last updated: 2014-06-19.

Intended use: Treatment of gamma sarcoglycanopathy

Source: https://www.ema.europa.eu/en/medicines/human/orphan-designations/eu-3-11-908